EMA orphan designation EU/3/15/1559
Active substance: 2-(2-chlorophenyl)-4-[3-(dimethylamino)phenyl]-5-methyl-1H-pyrazolo[4,3-C]pyridine-3,6(2H,5H)-dione
Designated 2015-10-09. Orphan designation status: Positive.
First published: 2015-11-18. Last updated: 2022-08-30.

Intended use: Treatment of systemic sclerosis

Source: https://www.ema.europa.eu/en/medicines/human/orphan-designations/eu-3-15-1559